EMA orphan designation EU/3/15/1555
Active substance: synthetic hepcidin
Designated 2015-10-09. Orphan designation status: Withdrawn.
First published: 2015-11-16. Last updated: 2022-05-18.

Intended use: Treatment of beta thalassaemia intermedia and major

Source: https://www.ema.europa.eu/en/medicines/human/orphan-designations/eu-3-15-1555